EMA orphan designation EU/3/09/719
Active substance: givinostat
Designated 2010-02-03. Orphan designation status: Positive.
First published: 2010-03-04. Last updated: 2010-03-04.

Intended use: Treatment of polycythaemia vera

Source: https://www.ema.europa.eu/en/medicines/human/orphan-designations/eu-3-09-719